EMA orphan designation EU/3/18/2008
Active substance: adeno-associated viral vector serotype 9 encoding miRNA against human superoxide dismutase 1
Designated 2018-04-16. Orphan designation status: Positive.
First published: 2018-05-17. Last updated: 2018-05-17.

Intended use: Treatment of amyotrophic lateral sclerosis

Source: https://www.ema.europa.eu/en/medicines/human/orphan-designations/eu-3-18-2008